EMA orphan designation EU/3/24/2976
Active substance: 4,9-Dimethyl-6-(4'-aminophenyl)-2H-furo[2,3-h]-1-benzopyran-2-one
Designated 2024-11-10. Orphan designation status: Positive.
First published: 2024-11-20.

Intended use: Treatment of cystic fibrosis

Source: https://www.ema.europa.eu/en/medicines/human/orphan-designations/eu-3-24-2976